EMA orphan designation EU/3/15/1574
Active substance: 4'-[(2-butyl-4-oxo-1,3-diazaspiro[4.4]non-1-en-3-yl)methyl]-N-(4,5-dimethyl-3-isoxazolyl)-2'-(ethoxymethyl)-[1,1'-biphenyl]-2-sulfonamide
Designated 2015-11-11. Orphan designation status: Positive.
First published: 2016-01-06. Last updated: 2022-01-12.

Intended use: Treatment of focal segmental glomerulosclerosis

Source: https://www.ema.europa.eu/en/medicines/human/orphan-designations/eu-3-15-1574